EMA orphan designation EU/3/11/874
Active substance: human embryonic stem-cell-derived retinal pigment epithelial cells
Designated 2011-06-21. Orphan designation status: Withdrawn.
First published: 2011-07-12. Last updated: 2023-07-19.

Intended use: Treatment of Stargardt's disease

Source: https://www.ema.europa.eu/en/medicines/human/orphan-designations/eu-3-11-874